EMA orphan designation EU/3/16/1723
Active substance: methotrexate
Designated 2016-08-29. Orphan designation status: Positive.
First published: 2016-10-03. Last updated: 2016-10-03.

Intended use: Treatment of alkaptonuria

Source: https://www.ema.europa.eu/en/medicines/human/orphan-designations/eu-3-16-1723